EMA orphan designation EU/3/09/677
Active substance: Human tumour necrosis factor alpha-derived peptide Cys-Gly-Gln-Arg-Glu-Thr-Pro-Glu-Gly-Ala-Glu-Ala-Lys-Pro-Trp-Tyr-Cys
Designated 2009-10-08. Orphan designation status: Positive.
First published: 2009-10-20. Last updated: 2009-10-20.

Intended use: Treatment of acute lung Injury

Source: https://www.ema.europa.eu/en/medicines/human/orphan-designations/eu-3-09-677